EMA orphan designation EU/3/01/081
Active substance: Fumagillin
Designated 2002-02-05. Orphan designation status: Withdrawn.
First published: 2007-05-30. Last updated: 2016-08-10.

Intended use: Treatment of diarrhoea associated with intestinal microsporidial infection

Source: https://www.ema.europa.eu/en/medicines/human/orphan-designations/eu-3-01-081